EMA orphan designation EU/3/17/1929
Active substance: recombinant monoclonal antibody to sialic acid-binding Ig-like lectin 8
Designated 2017-10-16. Orphan designation status: Withdrawn.
First published: 2018-01-08. Last updated: 2026-05-26.

Intended use: Treatment of mastocytosis

Source: https://www.ema.europa.eu/en/medicines/human/orphan-designations/eu-3-17-1929